EMA orphan designation EU/3/19/2149
Active substance: Adeno-associated viral vector serotype rh10 containing the human cholesterol 24-hydroxylase gene
Designated 2019-04-01. Orphan designation status: Withdrawn.
First published: 2019-05-07. Last updated: 2025-03-12.

Intended use: Treatment of Huntington’s disease

Source: https://www.ema.europa.eu/en/medicines/human/orphan-designations/eu-3-19-2149